EMA orphan designation EU/3/04/232
Active substance: biotinylated anti-tenascin monoclonal antibody
Designated 2004-10-20. Orphan designation status: Positive.
First published: 2006-01-04. Last updated: 2006-01-04.

Intended use: Treatment of glioma

Source: https://www.ema.europa.eu/en/medicines/human/orphan-designations/eu-3-04-232